EMA orphan designation EU/3/13/1131
Active substance: recombinant human CXCL8 mutant
Designated 2013-06-07. Orphan designation status: Positive.
First published: 2013-06-25. Last updated: 2013-06-25.

Intended use: Treatment of cystic fibrosis

Source: https://www.ema.europa.eu/en/medicines/human/orphan-designations/eu-3-13-1131